EMA orphan designation EU/3/25/3039
Active substance: single guide RNA containing a sequence complementary to human ALB locus gene, intron 1, target region, Ziclumeran
Designated 2025-03-25. Orphan designation status: Positive.
First published: 2025-04-07.

Intended use: Treatment of haemophilia B

Source: https://www.ema.europa.eu/en/medicines/human/orphan-designations/eu-3-25-3039